EMA orphan designation EU/3/16/1644
Active substance: brincidofovir
Designated 2016-04-28. Orphan designation status: Positive.
First published: 2016-05-30. Last updated: 2024-10-11.

Intended use: Prevention of cytomegalovirus disease

Source: https://www.ema.europa.eu/en/medicines/human/orphan-designations/eu-3-16-1644